EMA orphan designation EU/3/00/008
Active substance: arsenic trioxide
Designated 2000-10-18. Orphan designation status: Expired.
First published: 2007-07-04. Last updated: 2013-10-17.

Intended use: Treatment of acute promyelocytic leukaemia

Source: https://www.ema.europa.eu/en/medicines/human/orphan-designations/eu-3-00-008